EMA orphan designation EU/3/10/750
Active substance: rifapentine
Designated 2010-06-09. Orphan designation status: Positive.
First published: 2010-06-23. Last updated: 2023-06-12.

Intended use: Treatment of tuberculosis

Source: https://www.ema.europa.eu/en/medicines/human/orphan-designations/eu-3-10-750